EMA orphan designation EU/3/11/913
Active substance: resminostat
Designated 2011-09-27. Orphan designation status: Withdrawn.
First published: 2011-10-17. Last updated: 2025-06-05.

Intended use: Treatment of hepatocellular carcinoma

Source: https://www.ema.europa.eu/en/medicines/human/orphan-designations/eu-3-11-913